EMA orphan designation EU/3/22/2619
Active substance: chimeric peptide of human glucagon-like peptide-1, glucagon and gastric inhibitory polypeptide analogues linked to a human immunoglobulin Fc fragment
Designated 2022-05-16. Orphan designation status: Positive.
First published: 2022-12-14.

Intended use: Treatment of idiopathic pulmonary fibrosis

Source: https://www.ema.europa.eu/en/medicines/human/orphan-designations/eu-3-22-2619